EMA orphan designation EU/3/13/1106
Active substance: L-asparaginase encapsulated in erythrocytes
Designated 2013-02-08. Orphan designation status: Positive.
First published: 2013-03-04. Last updated: 2013-03-04.

Intended use: Treatment of acute myeloid leukaemia

Source: https://www.ema.europa.eu/en/medicines/human/orphan-designations/eu-3-13-1106